EMA orphan designation EU/3/08/553
Active substance: recombinant fusion protein of circulary-permuted IL-4 and pseudomonas exotoxin A, [IL-4(38-37)-PE38KDEL]
Designated 2008-06-03. Orphan designation status: Positive.
First published: 2008-08-18. Last updated: 2008-08-18.

Intended use: Treatment of glioma

Source: https://www.ema.europa.eu/en/medicines/human/orphan-designations/eu-3-08-553